EMA orphan designation EU/3/15/1476
Active substance: 1-(4-(N-glycylamido)phenyl)-3-trifluoromethyl-5-(phenanthren-2-yl)-pyrazole-hydrochloride
Designated 2015-04-24. Orphan designation status: Positive.
First published: 2015-05-21. Last updated: 2015-05-21.

Intended use: Treatment of tularaemia

Source: https://www.ema.europa.eu/en/medicines/human/orphan-designations/eu-3-15-1476